EMA orphan designation EU/3/08/544
Active substance: omigapil maleate
Designated 2008-05-08. Orphan designation status: Withdrawn.
First published: 2008-07-29. Last updated: 2025-01-15.

Intended use: Treatment of congenital muscular dystrophy with merosin (laminin alpha 2) deficiency

Source: https://www.ema.europa.eu/en/medicines/human/orphan-designations/eu-3-08-544